EMA orphan designation EU/3/25/3161
Active substance: humanised IgG1 kappa monoclonal antibody against pregnancy-associated plasma protein A
Designated 2025-09-12. Orphan designation status: Positive.
First published: 2026-03-26.

Intended use: Treatment of autosomal dominant polycystic kidney disease

Source: https://www.ema.europa.eu/en/medicines/human/orphan-designations/eu-3-25-3161